EMA orphan designation EU/3/03/139
Active substance: Bosentan
Designated 2003-03-18. Orphan designation status: Withdrawn.
First published: 2007-05-30. Last updated: 2014-05-08.

Intended use: Treatment of systemic sclerosis (scleroderma)

Source: https://www.ema.europa.eu/en/medicines/human/orphan-designations/eu-3-03-139